EMA orphan designation EU/3/25/3045
Active substance: nipocalimab
Orphan designation status: Positive.
First published: 2025-06-10.

Intended use: Prevention of fetal and neonatal alloimmune thrombocytopenia

Source: https://www.ema.europa.eu/en/medicines/human/orphan-designations/eu-3-25-3045